EMA orphan designation EU/3/09/623
Active substance: autologous haematopoietic stem cells transduced with lentiviral vector encoding the human beta-globin gene
Designated 2009-04-29. Orphan designation status: Positive.
First published: 2009-05-12. Last updated: 2023-03-24.

Intended use: Treatment of beta thalassaemia intermedia and major

Source: https://www.ema.europa.eu/en/medicines/human/orphan-designations/eu-3-09-623